EMA orphan designation EU/3/15/1486
Active substance: recombinant human mesencephalic astrocyte-derived neurotrophic factor
Designated 2015-04-24. Orphan designation status: Positive.
First published: 2015-05-22. Last updated: 2023-01-24.

Intended use: Treatment of retinitis pigmentosa

Source: https://www.ema.europa.eu/en/medicines/human/orphan-designations/eu-3-15-1486